EMA orphan designation EU/3/09/639
Active substance: 4,6,8-Trihydroxy-10-(3,7,11-trimethyldodeca-2,6,10-trienyl)-5,10-dihydrodibenzo[b,e][1,4] diazepin-11-one
Designated 2009-05-15. Orphan designation status: Withdrawn.
First published: 2009-06-09. Last updated: 2013-10-17.

Intended use: Treatment of glioma

Source: https://www.ema.europa.eu/en/medicines/human/orphan-designations/eu-3-09-639